EMA orphan designation EU/3/12/1006
Active substance: Doxorubicin
Designated 2012-11-28. Orphan designation status: Withdrawn.
First published: 2012-12-17. Last updated: 2017-05-17.

Intended use: Treatment of glioma

Source: https://www.ema.europa.eu/en/medicines/human/orphan-designations/eu-3-12-1006